EMA orphan designation EU/3/21/2430
Active substance: elamipretide
Designated 2021-05-20. Orphan designation status: Positive.
First published: 2022-03-17. Last updated: 2024-10-02.

Intended use: Treatment of Barth syndrome

Source: https://www.ema.europa.eu/en/medicines/human/orphan-designations/eu-3-21-2430